EMA orphan designation EU/3/08/530
Active substance: heterologous human adult liver derived stem cells
Designated 2008-02-04. Orphan designation status: Positive.
First published: 2008-07-10. Last updated: 2024-10-15.

Intended use: Treatment of ornithinine transcarbamylase deficiency

Source: https://www.ema.europa.eu/en/medicines/human/orphan-designations/eu-3-08-530